EMA orphan designation EU/3/14/1429
Active substance: N-methyl-4-({4-[({3-methyl(methylsulfonyl)aminopyrazin-2-yl}methyl)amino]-5-(trifluoromethyl)pyrimidin-2-yl}amino)benzamide hydrochloride (defactinib)
Designated 2015-01-15. Orphan designation status: Positive.
First published: 2015-03-06. Last updated: 2022-05-24.

Intended use: Treatment of ovarian cancer

Source: https://www.ema.europa.eu/en/medicines/human/orphan-designations/eu-3-14-1429